EMA orphan designation EU/3/08/531
Active substance: ascorbic acid
Designated 2008-04-01. Orphan designation status: Positive.
First published: 2008-07-10. Last updated: 2014-04-01.

Intended use: Treatment of Charcot-Marie-Tooth disease type 1A

Source: https://www.ema.europa.eu/en/medicines/human/orphan-designations/eu-3-08-531